EMA orphan designation EU/3/23/2790
Active substance: autologous dendritic cells pulsed with allogeneic tumour cell lysate
Designated 2023-06-20. Orphan designation status: Positive.
First published: 2024-02-02.

Intended use: Treatment of pancreatic cancer

Source: https://www.ema.europa.eu/en/medicines/human/orphan-designations/eu-3-23-2790